EMA orphan designation EU/3/09/673
Active substance: recombinant antibody construct against human CD30 and CD16A
Designated 2009-10-09. Orphan designation status: Positive.
First published: 2009-10-20. Last updated: 2016-08-17.

Intended use: Treatment of Hodgkin's lymphoma

Source: https://www.ema.europa.eu/en/medicines/human/orphan-designations/eu-3-09-673